EMA orphan designation EU/3/09/613
Active substance: tobramycin
Designated 2009-02-28. Orphan designation status: Withdrawn.
First published: 2009-06-29. Last updated: 2018-02-09.

Intended use: Treatment of Pseudomonas aeruginosa lung infection in cystic fibrosis

Source: https://www.ema.europa.eu/en/medicines/human/orphan-designations/eu-3-09-613